EMA orphan designation EU/3/10/746
Active substance: Maytansinoid-conjugated humanised monoclonal antibody against CD56
Designated 2010-06-09. Orphan designation status: Withdrawn.
First published: 2010-06-23. Last updated: 2015-03-06.

Intended use: Treatment of Merkel cell carcinoma

Source: https://www.ema.europa.eu/en/medicines/human/orphan-designations/eu-3-10-746